EMA orphan designation EU/3/13/1127: Besponsa
Active substance: Inotuzumab ozogamicin
Designated 2013-06-07. Orphan designation status: Positive. EMA product number: EMEA/H/C/004119.
First published: 2013-06-25. Last updated: 2013-06-25.

Intended use: Treatment of B-cell acute lymphoblastic leukaemia

Source: https://www.ema.europa.eu/en/medicines/human/orphan-designations/eu-3-13-1127